EMA orphan designation EU/3/01/076
Active substance: Octavalent Pseudomonas aeruginosa O-polysaccharide-toxin A conjugate
Designated 2001-12-11. Orphan designation status: Withdrawn.
First published: 2009-05-15. Last updated: 2009-05-15.

Intended use: Prevention of Pseudomonas aeruginosa infections in patients with cystic fibrosis

Source: https://www.ema.europa.eu/en/medicines/human/orphan-designations/eu-3-01-076